EMA orphan designation EU/3/01/027
Active substance: Ibuprofen
Designated 2001-03-06. Orphan designation status: Withdrawn.
First published: 2009-05-15. Last updated: 2009-05-15.

Intended use: Patent ductus arteriosus in premature neonates of less than 34 weeks of gestational age

Source: https://www.ema.europa.eu/en/medicines/human/orphan-designations/eu-3-01-027